EMA orphan designation EU/3/14/1325
Active substance: obinutuzumab
Designated 2014-08-22. Orphan designation status: Withdrawn.
First published: 2014-10-02. Last updated: 2019-02-21.

Intended use: Treatment of diffuse large B-cell lymphoma

Source: https://www.ema.europa.eu/en/medicines/human/orphan-designations/eu-3-14-1325